EMA orphan designation EU/3/01/061
Active substance: imatinib mesilate
Designated 2001-11-20. Orphan designation status: Expired.
First published: 2005-11-29. Last updated: 2015-02-09.

Intended use: Treatment of malignant gastrointestinal stromal tumours

Source: https://www.ema.europa.eu/en/medicines/human/orphan-designations/eu-3-01-061